EMA orphan designation EU/3/09/627
Active substance: Lintuzumab
Designated 2009-04-30. Orphan designation status: Withdrawn.
First published: 2009-05-12. Last updated: 2011-11-11.

Intended use: Treatment of acute myeloid leukaemia

Source: https://www.ema.europa.eu/en/medicines/human/orphan-designations/eu-3-09-627